EMA orphan designation EU/3/23/2773
Active substance: Escherichia coli, strain Nissle 1917, expressing high affinity phenylalanine transporter, phenylalanine ammonia lyase and L-amino acid deaminase
Designated 2023-04-21. Orphan designation status: Positive.
First published: 2024-01-25.

Intended use: Treatment of hyperphenylalaninaemia

Source: https://www.ema.europa.eu/en/medicines/human/orphan-designations/eu-3-23-2773